EMA orphan designation EU/3/01/024
Active substance: ribavirin
Designated 2001-02-14. Orphan designation status: Withdrawn.
First published: 2009-03-03. Last updated: 2011-05-07.

Intended use: Treatment of haemorrhagic fever with renal syndrome

Source: https://www.ema.europa.eu/en/medicines/human/orphan-designations/eu-3-01-024